EMA orphan designation EU/3/13/1148
Active substance: autologous bone-marrow-derived mesenchymal stromal cells secreting neurotrophic factors
Designated 2013-07-17. Orphan designation status: Positive.
First published: 2013-08-16. Last updated: 2013-08-16.

Intended use: Treatment of amyotrophic lateral sclerosis

Source: https://www.ema.europa.eu/en/medicines/human/orphan-designations/eu-3-13-1148